EMA orphan designation EU/3/17/1893
Active substance: Polyphenyl(disodium 3-O-sulfo-beta-D-glucopyranuronate)-(1->3)-beta-D-galactopyranoside
Designated 2017-07-17. Orphan designation status: Withdrawn.
First published: 2017-08-16. Last updated: 2021-11-15.

Intended use: Treatment of anti-MAG neuropathy

Source: https://www.ema.europa.eu/en/medicines/human/orphan-designations/eu-3-17-1893